EMA orphan designation EU/3/22/2649: Ordspono
Active substance: odronextamab
Designated 2022-07-18. Orphan designation status: Withdrawn. EMA product number: EMEA/H/C/006215.
First published: 2023-01-25. Last updated: 2024-06-28.

Intended use: Treatment of follicular lymphoma

Source: https://www.ema.europa.eu/en/medicines/human/orphan-designations/eu-3-22-2649